EMA orphan designation EU/3/08/548
Active substance: carfilzomib
Designated 2008-06-03. Orphan designation status: Expired.
First published: 2009-09-17. Last updated: 2025-11-28.

Intended use: Treatment of multiple myeloma

Source: https://www.ema.europa.eu/en/medicines/human/orphan-designations/eu-3-08-548